EMA orphan designation EU/3/17/1890
Active substance: autologous CD4+ and CD8+ T cells expressing a CD19-specific chimeric antigen receptor (lisocabtagene maraleucel)
Designated 2017-07-17. Orphan designation status: Withdrawn.
First published: 2017-08-14. Last updated: 2022-05-04.

Intended use: Treatment of diffuse large B-cell lymphoma

Source: https://www.ema.europa.eu/en/medicines/human/orphan-designations/eu-3-17-1890